EMA orphan designation EU/3/12/1043: Folcepri
Active substance: N-[4-[[(2-amino-3,4-dihydro-4-oxo-6-pteridinyl)methyl]amino]benzoyl]-D-gamma-glutamyl-(2S)-2-amino-beta-alanyl-L-alpha-aspartyl-L-cysteine (etarfolatide)
Designated 2012-09-10. Orphan designation status: Withdrawn. EMA product number: EMEA/H/C/002570.
First published: 2012-09-27. Last updated: 2021-12-07.

Intended use: Diagnosis of positive folate-receptor status in ovarian cancer

Source: https://www.ema.europa.eu/en/medicines/human/orphan-designations/eu-3-12-1043